EMA orphan designation EU/3/16/1827
Active substance: trans-resveratrol
Designated 2017-01-12. Orphan designation status: Positive.
First published: 2017-07-13. Last updated: 2017-07-13.

Intended use: Treatment of spinocerebellar ataxia

Source: https://www.ema.europa.eu/en/medicines/human/orphan-designations/eu-3-16-1827